EMA orphan designation EU/3/03/135
Active substance: decitabine
Designated 2003-02-14. Orphan designation status: Positive.
First published: 2007-05-30. Last updated: 2007-05-30.

Intended use: Treatment of myelodysplastic syndromes

Source: https://www.ema.europa.eu/en/medicines/human/orphan-designations/eu-3-03-135